EMA orphan designation EU/3/17/1944
Active substance: tamoxifen citrate
Designated 2017-10-12. Orphan designation status: Positive.
First published: 2018-01-15. Last updated: 2019-07-30.

Intended use: Treatment of Duchenne muscular dystrophy

Source: https://www.ema.europa.eu/en/medicines/human/orphan-designations/eu-3-17-1944